EMA orphan designation EU/3/25/3033
Active substance: adeno-associated virus serotype rh.10 containing the human PKP2 gene
Designated 2025-02-26. Orphan designation status: Positive.
First published: 2025-03-11.

Intended use: Treatment of arrhythmogenic cardiomyopathy caused by pathogenic mutations in the PKP2 gene

Source: https://www.ema.europa.eu/en/medicines/human/orphan-designations/eu-3-25-3033